EMA orphan designation EU/3/20/2338
Active substance: decitabine; tetrahydrouridine
Designated 2020-10-19. Orphan designation status: Positive.
First published: 2021-02-15.

Intended use: Treatment of sickle cell disease

Source: https://www.ema.europa.eu/en/medicines/human/orphan-designations/eu-3-20-2338